EMA orphan designation EU/3/13/1196
Active substance: autologous CD34+ cells transfected with lentiviral vector containing the Wiskott-Aldrich syndrome protein gene (etuvetidigene autotemcel)
Designated 2013-10-07. Orphan designation status: Withdrawn.
First published: 2013-10-31. Last updated: 2025-02-20.

Intended use: Treatment of Wiskott-Aldrich syndrome

Source: https://www.ema.europa.eu/en/medicines/human/orphan-designations/eu-3-13-1196